EMA orphan designation EU/3/15/1541
Active substance: Ibrutinib
Designated 2015-08-10. Orphan designation status: Withdrawn.
First published: 2015-10-02. Last updated: 2021-11-23.

Intended use: Treatment of marginal zone lymphoma

Source: https://www.ema.europa.eu/en/medicines/human/orphan-designations/eu-3-15-1541